EMA orphan designation EU/3/05/271
Active substance: Paromomycin sulfate
Designated 2005-04-11. Orphan designation status: Withdrawn.
First published: 2005-07-01. Last updated: 2011-05-18.

Intended use: Treatment of visceral leishmaniasis

Source: https://www.ema.europa.eu/en/medicines/human/orphan-designations/eu-3-05-271